EMA orphan designation EU/3/15/1449
Active substance: myriocin
Designated 2015-02-12. Orphan designation status: Positive.
First published: 2015-04-09. Last updated: 2021-10-13.

Intended use: Treatment of retinitis pigmentosa

Source: https://www.ema.europa.eu/en/medicines/human/orphan-designations/eu-3-15-1449